EMA orphan designation EU/3/13/1142
Active substance: adenovirus associated viral vector serotype 5 containing the human pde6β gene
Designated 2013-06-19. Orphan designation status: Positive.
First published: 2013-07-11. Last updated: 2024-10-11.

Intended use: Treatment of retinitis pigmentosa

Source: https://www.ema.europa.eu/en/medicines/human/orphan-designations/eu-3-13-1142